EMA orphan designation EU/3/23/2772
Active substance: autologous adipose-derived mesenchymal stem cells
Designated 2023-04-21. Orphan designation status: Positive.
First published: 2024-02-01.

Intended use: Treatment of oesophageal atresia

Source: https://www.ema.europa.eu/en/medicines/human/orphan-designations/eu-3-23-2772